EMA orphan designation EU/3/17/1894
Active substance: recombinant human antibody directed against misfolded human superoxide dismutase 1
Designated 2017-07-17. Orphan designation status: Positive.
First published: 2017-08-14. Last updated: 2017-08-14.

Intended use: Treatment of amyotrophic lateral sclerosis

Source: https://www.ema.europa.eu/en/medicines/human/orphan-designations/eu-3-17-1894